EMA orphan designation EU/3/10/736: Ravicti
Active substance: Glyceryl tri-(4-phenylbutyrate)
Designated 2010-06-10. Orphan designation status: Positive. EMA product number: EMEA/H/C/003822.
First published: 2010-06-24. Last updated: 2022-11-18.

Intended use: Treatment of argininosuccinic aciduria

Source: https://www.ema.europa.eu/en/medicines/human/orphan-designations/eu-3-10-736